EMA orphan designation EU/3/11/945
Active substance: ornithine phenylacetate
Orphan designation status: Positive.
First published: 2012-02-01. Last updated: 2025-03-12.

Intended use: Treatment of acute liver failure

Source: https://www.ema.europa.eu/en/medicines/human/orphan-designations/eu-3-11-945